EMA orphan designation EU/3/15/1563
Active substance: recombinant human interleukin-3 truncated diphtheria toxin fusion protein
Designated 2015-10-09. Orphan designation status: Positive.
First published: 2015-11-18. Last updated: 2022-04-25.

Intended use: Treatment of acute myeloid leukaemia

Source: https://www.ema.europa.eu/en/medicines/human/orphan-designations/eu-3-15-1563